EMA orphan designation EU/3/10/772
Active substance: adenovirus-associated viral vector serotype 10 carrying the human N-sulfoglucosamine sulfohydrolase and sulfatase modifying factor 1 cDNAs
Designated 2010-09-20. Orphan designation status: Positive.
First published: 2010-10-18. Last updated: 2015-05-13.

Intended use: Treatment of mucopolysaccharidosis, type IIIA (Sanfilippo A syndrome)

Source: https://www.ema.europa.eu/en/medicines/human/orphan-designations/eu-3-10-772